EMA orphan designation EU/3/25/3183
Active substance: deupirfenidone
Designated 2026-01-09. Orphan designation status: Positive.
First published: 2026-03-25.

Intended use: Treatment idiopathic pulmonary fibrosis

Source: https://www.ema.europa.eu/en/medicines/human/orphan-designations/eu-3-25-3183